EMA orphan designation EU/3/25/3156
Active substance: Felzartamab
Designated 2025-11-21. Orphan designation status: Positive.
First published: 2026-04-14.

Intended use: Treatment of primary membranous nephropathy

Source: https://www.ema.europa.eu/en/medicines/human/orphan-designations/eu-3-25-3156